EMA orphan designation EU/3/18/1986
Active substance: seletalisib
Designated 2018-02-22. Orphan designation status: Withdrawn.
First published: 2018-04-11. Last updated: 2021-10-19.

Intended use: Treatment of activated phosphoinositide 3-kinase delta syndrome

Source: https://www.ema.europa.eu/en/medicines/human/orphan-designations/eu-3-18-1986